EMA orphan designation EU/3/13/1177
Active substance: chimaeric monoclonal antibody against claudin-18 splice variant 2
Designated 2013-08-05. Orphan designation status: Positive.
First published: 2013-09-13. Last updated: 2017-06-08.

Intended use: Treatment of pancreatic cancer

Source: https://www.ema.europa.eu/en/medicines/human/orphan-designations/eu-3-13-1177